EMA orphan designation EU/3/18/1993
Active substance: Gemfibrozil
Designated 2018-03-21. Orphan designation status: Positive.
First published: 2018-05-14. Last updated: 2019-05-22.

Intended use: Treatment of neuronal ceroid lipofuscinosis

Source: https://www.ema.europa.eu/en/medicines/human/orphan-designations/eu-3-18-1993